EMA orphan designation EU/3/18/1995
Active substance: Larotrectinib
Designated 2018-03-21. Orphan designation status: Withdrawn.
First published: 2018-05-08. Last updated: 2019-10-24.

Intended use: Treatment of salivary gland cancer

Source: https://www.ema.europa.eu/en/medicines/human/orphan-designations/eu-3-18-1995